EMA orphan designation EU/3/22/2586
Active substance: codergocrine mesilate; oxitriptan
Designated 2022-03-16. Orphan designation status: Positive.
First published: 2022-06-16.

Intended use: Treatment of fragile X syndrome

Source: https://www.ema.europa.eu/en/medicines/human/orphan-designations/eu-3-22-2586